EMA orphan designation EU/3/21/2402
Active substance: 2-(2-{[2-(1h-benzimidazol-2-yl)ethyl]amino}ethyl)-n-[(3-fluoropyridin-2-yl)methyl]-1,3-oxazole-4-carboxamide trihydrochloride
Designated 2021-02-19. Orphan designation status: Positive.
First published: 2022-02-22.

Intended use: Treatment of sickle cell disease

Source: https://www.ema.europa.eu/en/medicines/human/orphan-designations/eu-3-21-2402